EMA orphan designation EU/3/24/2971
Active substance: adeno-associated viral vector serotype 3B encoding human CYP27A1
Orphan designation status: Positive.
First published: 2024-09-19.

Intended use: Treatment of inborn errors in primary bile acid synthesis

Source: https://www.ema.europa.eu/en/medicines/human/orphan-designations/eu-3-24-2971